EMA orphan designation EU/3/05/266
Active substance: ciclosporin
Designated 2005-03-10. Orphan designation status: Withdrawn.
First published: 2007-12-04. Last updated: 2016-10-21.

Intended use: Prevention of graft rejection after lung transplantation

Source: https://www.ema.europa.eu/en/medicines/human/orphan-designations/eu-3-05-266